EMA orphan designation EU/3/09/723: Idelvion
Active substance: recombinant fusion protein linking human coagulation factor IX with human albumin
Designated 2010-02-04. Orphan designation status: Positive. EMA product number: EMEA/H/C/003955.
First published: 2010-03-04. Last updated: 2018-03-28.

Intended use: Treatment of haemophilia B

Source: https://www.ema.europa.eu/en/medicines/human/orphan-designations/eu-3-09-723